EMA orphan designation EU/3/07/478
Active substance: N-(2-amino-phenyl)-4-[(4-pyridin-3-yl-pyrimidin-2-ylamino)-methyl] benzamide (mocetinostat)
Designated 2007-09-14. Orphan designation status: Withdrawn.
First published: 2009-09-23. Last updated: 2022-05-10.

Intended use: Treatment of Hodgkin's lymphoma

Source: https://www.ema.europa.eu/en/medicines/human/orphan-designations/eu-3-07-478